EMA orphan designation EU/3/07/484
Active substance: adenovirus associated viral vector serotype 4 containing the human RPE65 gene
Designated 2007-10-22. Orphan designation status: Positive.
First published: 2008-01-22. Last updated: 2022-08-09.

Intended use: Treatment of Leber's congenital amaurosis

Source: https://www.ema.europa.eu/en/medicines/human/orphan-designations/eu-3-07-484